EMA orphan designation EU/3/18/1997
Active substance: Miransertib
Designated 2018-03-21. Orphan designation status: Positive.
First published: 2018-05-15. Last updated: 2020-12-22.

Intended use: Treatment of Proteus syndrome

Source: https://www.ema.europa.eu/en/medicines/human/orphan-designations/eu-3-18-1997